EMA orphan designation EU/3/09/695
Active substance: 8-[4-(1-aminocyclobutyl)phenyl]-9-phenyl-1,2,4-triazolo[3,4-f][1,6]naphthyridin-3(2H)-one mono-hydrochloride
Designated 2009-11-30. Orphan designation status: Withdrawn.
First published: 2009-12-10. Last updated: 2019-02-21.

Intended use: Treatment of ovarian cancer

Source: https://www.ema.europa.eu/en/medicines/human/orphan-designations/eu-3-09-695